EMA orphan designation EU/3/17/1838
Active substance: Humanised IgG4 monoclonal antibody to the human toll-like receptor type 2
Designated 2017-02-27. Orphan designation status: Positive.
First published: 2017-03-13. Last updated: 2017-03-13.

Intended use: Treatment of pancreatic cancer

Source: https://www.ema.europa.eu/en/medicines/human/orphan-designations/eu-3-17-1838